EMA orphan designation EU/3/17/1934
Active substance: (R)-troloxamide quinone
Designated 2017-10-12. Orphan designation status: Positive.
First published: 2018-01-15. Last updated: 2019-12-03.

Intended use: Treatment of amyotrophic lateral sclerosis

Source: https://www.ema.europa.eu/en/medicines/human/orphan-designations/eu-3-17-1934